EMA orphan designation EU/3/16/1824
Active substance: Pr-D-Cys-Met-Pip-Arg-Leu-Arg-Sar-Cys-Lys-Arg-Pro-Tyr-Tle-Leu-OH
Designated 2017-01-12. Orphan designation status: Positive.
First published: 2017-07-13. Last updated: 2017-07-13.

Intended use: Treatment of perinatal asphyxia

Source: https://www.ema.europa.eu/en/medicines/human/orphan-designations/eu-3-16-1824